EMA orphan designation EU/3/16/1707: Prohippur
Active substance: sodium benzoate
Designated 2016-07-14. Orphan designation status: Positive. EMA product number: EMEA/H/C/004150.
First published: 2016-09-06. Last updated: 2016-09-06.

Intended use: Treatment of citrullinaemia type 1

Source: https://www.ema.europa.eu/en/medicines/human/orphan-designations/eu-3-16-1707